EMA orphan designation EU/3/17/1842
Active substance: recombinant human club cell 10 KDa protein
Designated 2017-02-27. Orphan designation status: Positive.
First published: 2017-03-31. Last updated: 2019-04-08.

Intended use: Treatment of bronchiolitis obliterans syndrome

Source: https://www.ema.europa.eu/en/medicines/human/orphan-designations/eu-3-17-1842